EMA orphan designation EU/3/08/591
Active substance: 5-(Ethylsulfonyl)-2-(naphthalen-2-yl)benzo[d]oxazole (ezutromid)
Designated 2008-12-04. Orphan designation status: Withdrawn.
First published: 2009-06-29. Last updated: 2021-02-10.

Intended use: Treatment of Duchenne muscular dystrophy

Source: https://www.ema.europa.eu/en/medicines/human/orphan-designations/eu-3-08-591